EMA orphan designation EU/3/22/2611
Active substance: Fusion protein composed of the first 2 immunoglobulin-like domains of the human Roundabout guidance receptor 2 fused to a human IgG1 crystallised fragment
Designated 2022-05-16. Orphan designation status: Withdrawn.
First published: 2022-12-14. Last updated: 2023-07-27.

Intended use: Treatment of focal segmental glomerulosclerosis

Source: https://www.ema.europa.eu/en/medicines/human/orphan-designations/eu-3-22-2611